EMA orphan designation EU/3/18/2088
Active substance: Ex vivo fused normal allogeneic human myoblast with another normal allogeneic human myoblast
Designated 2018-11-19. Orphan designation status: Positive.
First published: 2019-02-25. Last updated: 2023-04-28.

Intended use: Treatment of Duchenne muscular dystrophy

Source: https://www.ema.europa.eu/en/medicines/human/orphan-designations/eu-3-18-2088